EMA orphan designation EU/3/04/243
Active substance: alpha-1 antitrypsin
Designated 2004-11-16. Orphan designation status: Positive.
First published: 2009-09-17. Last updated: 2019-04-25.

Intended use: Treatment of cystic fibrosis

Source: https://www.ema.europa.eu/en/medicines/human/orphan-designations/eu-3-04-243